EMA orphan designation EU/3/15/1522
Active substance: Humanised IgG4 monoclonal antibody against extracellular tau
Designated 2015-07-28. Orphan designation status: Positive.
First published: 2015-08-18. Last updated: 2019-04-29.

Intended use: Treatment of progressive supranuclear palsy

Source: https://www.ema.europa.eu/en/medicines/human/orphan-designations/eu-3-15-1522